EMA orphan designation EU/3/15/1595
Active substance: live attenuated Listeria monocytogenes bioengineered with a chimeric human epidermal growth factor receptor 2 fused to a truncated form of the Lm protein listeriolysin O
Designated 2015-12-14. Orphan designation status: Positive.
First published: 2016-02-03. Last updated: 2018-05-03.

Intended use: Treatment of osteosarcoma

Source: https://www.ema.europa.eu/en/medicines/human/orphan-designations/eu-3-15-1595